EMA orphan designation EU/3/20/2323
Active substance: trehalose
Designated 2020-08-21. Orphan designation status: Positive.
First published: 2020-11-25.

Intended use: Treatment of mucopolysaccharidosis type III (Sanfilippo syndrome)

Source: https://www.ema.europa.eu/en/medicines/human/orphan-designations/eu-3-20-2323